EMA orphan designation EU/3/14/1406: Chenodeoxycholic acid Leadiant (previously Chenodeoxycholic acid sigma-tau)
Active substance: chenodeoxycholic acid
Designated 2014-12-16. Orphan designation status: Positive. EMA product number: EMEA/H/C/004061.
First published: 2015-02-27. Last updated: 2017-06-29.

Intended use: Treatment of inborn errors in primary bile acid synthesis

Source: https://www.ema.europa.eu/en/medicines/human/orphan-designations/eu-3-14-1406